EMA orphan designation EU/3/11/865
Active substance: 9-cis-Retinyl acetate
Designated 2011-05-13. Orphan designation status: Positive.
First published: 2011-05-27. Last updated: 2019-05-13.

Intended use: Treatment of retinitis pigmentosa

Source: https://www.ema.europa.eu/en/medicines/human/orphan-designations/eu-3-11-865